EMA orphan designation EU/3/11/932
Active substance: pegylated proline-interferon alpha-2b (ropeginterferon alfa-2b)
Designated 2011-12-09. Orphan designation status: Withdrawn.
First published: 2011-12-20. Last updated: 2019-05-08.

Intended use: Treatment of polycythaemia vera

Source: https://www.ema.europa.eu/en/medicines/human/orphan-designations/eu-3-11-932